EMA orphan designation EU/3/14/1358
Active substance: recombinant human pentraxin-2
Designated 2014-11-19. Orphan designation status: Positive.
First published: 2015-01-15. Last updated: 2020-06-08.

Intended use: Treatment of post-essential thrombocythaemia myelofibrosis

Source: https://www.ema.europa.eu/en/medicines/human/orphan-designations/eu-3-14-1358